EMA orphan designation EU/3/16/1791
Active substance: vaccine consisting of 5 survivin peptides with different human leukocyte antigen restrictions
Designated 2016-11-18. Orphan designation status: Positive.
First published: 2016-12-14. Last updated: 2020-06-08.

Intended use: Treatment of ovarian cancer

Source: https://www.ema.europa.eu/en/medicines/human/orphan-designations/eu-3-16-1791